EMA orphan designation EU/3/20/2386
Active substance: sodium oxybate; calcium oxybate; magnesium oxybate; potassium oxybate
Designated 2021-01-06. Orphan designation status: Positive.
First published: 2021-05-18.

Intended use: Treatment of idiopathic hypersomnia

Source: https://www.ema.europa.eu/en/medicines/human/orphan-designations/eu-3-20-2386